EMA orphan designation EU/3/21/2495
Active substance: lurbinectedin
Designated 2021-08-20. Orphan designation status: Positive.
First published: 2022-03-04.

Intended use: Treatment of malignant mesothelioma

Source: https://www.ema.europa.eu/en/medicines/human/orphan-designations/eu-3-21-2495